EMA orphan designation EU/3/18/2060
Active substance: adeno-associated viral vector serotype 2 containing the human REP1 gene (timrepigene emparvovec)
Designated 2018-08-24. Orphan designation status: Withdrawn.
First published: 2018-12-19. Last updated: 2021-01-07.

Intended use: Treatment of spinal muscular atrophy

Source: https://www.ema.europa.eu/en/medicines/human/orphan-designations/eu-3-18-2060